EMA orphan designation EU/3/18/2102
Active substance: apraglutide
Designated 2018-11-19. Orphan designation status: Positive.
First published: 2019-02-19.

Intended use: Treatment of short bowel syndrome

Source: https://www.ema.europa.eu/en/medicines/human/orphan-designations/eu-3-18-2102